EMA orphan designation EU/3/15/1529
Active substance: Glycyl-L-2-methylprolyl-L-glutamic acid
Designated 2015-07-28. Orphan designation status: Positive.
First published: 2015-08-18. Last updated: 2024-04-08.

Intended use: Treatment of fragile X syndrome

Source: https://www.ema.europa.eu/en/medicines/human/orphan-designations/eu-3-15-1529